EMA orphan designation EU/3/15/1492
Active substance: AASSGVSTPGSAGHDIITEQPRS
Designated 2015-05-12. Orphan designation status: Positive.
First published: 2015-06-30. Last updated: 2015-06-30.

Intended use: Treatment of Huntington’s disease

Source: https://www.ema.europa.eu/en/medicines/human/orphan-designations/eu-3-15-1492